EMA orphan designation EU/3/16/1772
Active substance: adeno-associated viral vector serotype 8 containing the human UGT1A1 gene (volrubigene ralaparvovec)
Designated 2016-11-18. Orphan designation status: Withdrawn.
First published: 2016-12-13. Last updated: 2021-02-03.

Intended use: Treatment of Crigler-Najjar syndrome

Source: https://www.ema.europa.eu/en/medicines/human/orphan-designations/eu-3-16-1772